EMA orphan designation EU/3/14/1262
Active substance: recombinant human surfactant protein D
Designated 2014-04-11. Orphan designation status: Positive.
First published: 2014-06-05. Last updated: 2022-03-18.

Intended use: Prevention of bronchopulmonary dysplasia

Source: https://www.ema.europa.eu/en/medicines/human/orphan-designations/eu-3-14-1262